EMA orphan designation EU/3/06/352
Active substance: 26 base single stranded phosphodiester DNA oligonucleotide
Designated 2006-02-16. Orphan designation status: Withdrawn.
First published: 2009-09-17. Last updated: 2011-05-18.

Intended use: Treatment of pancreatic cancer

Source: https://www.ema.europa.eu/en/medicines/human/orphan-designations/eu-3-06-352